EMA orphan designation EU/3/19/2200
Active substance: clofazimine
Designated 2019-08-21. Orphan designation status: Positive.
First published: 2020-01-16. Last updated: 2023-03-13.

Intended use: Treatment of nontuberculous mycobacterial lung disease

Source: https://www.ema.europa.eu/en/medicines/human/orphan-designations/eu-3-19-2200